EMA orphan designation EU/3/23/2783
Active substance: govorestat
Designated 2023-05-22. Orphan designation status: Positive.
First published: 2024-02-02.

Intended use: Treatment of Charcot-Marie-Tooth disease

Source: https://www.ema.europa.eu/en/medicines/human/orphan-designations/eu-3-23-2783